EMA orphan designation EU/3/25/3172
Active substance: biotin
Designated 2025-09-12. Orphan designation status: Positive.
First published: 2026-03-26.

Intended use: Treatment of biotin-thiamine-responsive basal ganglia disease (BTBGD)

Source: https://www.ema.europa.eu/en/medicines/human/orphan-designations/eu-3-25-3172